EMA orphan designation EU/3/02/100
Active substance: recombinant human alpha-1 antitrypsin
Designated 2002-04-30. Orphan designation status: Withdrawn.
First published: 2012-06-04. Last updated: 2014-01-13.

Intended use: Treatment of emphysema secondary to congenital alpha-1 antitrypsin deficiency

Source: https://www.ema.europa.eu/en/medicines/human/orphan-designations/eu-3-02-100